EMA orphan designation EU/3/16/1614
Active substance: tolfenamic acid
Designated 2016-02-17. Orphan designation status: Positive.
First published: 2016-04-07. Last updated: 2016-04-07.

Intended use: treatment of behavioural variant frontotemporal dementia

Source: https://www.ema.europa.eu/en/medicines/human/orphan-designations/eu-3-16-1614